EMA orphan designation EU/3/01/025
Active substance: N-acetylgalactosamine 4-sulfatase
Designated 2001-02-15. Orphan designation status: Expired.
First published: 2009-02-20. Last updated: 2016-02-10.

Intended use: Treatment of mucopolysaccharidosis VI (Maroteaux-Lamy syndrome)

Source: https://www.ema.europa.eu/en/medicines/human/orphan-designations/eu-3-01-025